EMA orphan designation EU/3/26/3214
Active substance: acetylleucine
Designated 2026-03-25. Orphan designation status: Positive.
First published: 2026-04-22.

Intended use: Treatment of CACNA1A disorders

Source: https://www.ema.europa.eu/en/medicines/human/orphan-designations/eu-3-26-3214